EMA orphan designation EU/3/25/3164
Active substance: human IgG1 monoclonal antibody against tumor necrosis factor receptor superfamily member 1B
Designated 2025-09-12. Orphan designation status: Positive.
First published: 2026-03-26.

Intended use: Treatment of cutaneous T-cell lymphoma

Source: https://www.ema.europa.eu/en/medicines/human/orphan-designations/eu-3-25-3164